EMA orphan designation EU/3/14/1335
Active substance: (S)-2-(1-((6-amino-5-cyanopyrimidin-4-yl)amino)ethyl)-4-oxo-3-phenyl-3,4-dihydropyrrolo[2,1-f][1,2,4]triazine-5-carbonitrile (tanziertib)
Designated 2014-10-15. Orphan designation status: Withdrawn.
First published: 2014-11-12. Last updated: 2018-02-22.

Intended use: Treatment of pemphigus

Source: https://www.ema.europa.eu/en/medicines/human/orphan-designations/eu-3-14-1335